EMA orphan designation EU/3/08/536
Active substance: chimeric antibody to mesothelin
Designated 2008-03-17. Orphan designation status: Withdrawn.
First published: 2008-07-10. Last updated: 2016-08-11.

Intended use: Treatment of pancreatic cancer

Source: https://www.ema.europa.eu/en/medicines/human/orphan-designations/eu-3-08-536